EMA orphan designation EU/3/25/3073
Active substance: Alpha-N-acetyl glucosaminidase fused to a humanised monoclonal antibody against transferrin receptor
Designated 2025-06-20. Orphan designation status: Positive.
First published: 2025-07-25.

Intended use: Treatment of mucopolysaccharidosis type IIIB (Sanfilippo B syndrome)

Source: https://www.ema.europa.eu/en/medicines/human/orphan-designations/eu-3-25-3073